EMA orphan designation EU/3/22/2580
Active substance: autologous CD34+ cells edited with CRISPR/Cas9 and transduced with an adeno-associated virus vector serotype 6 containing the codon-optimized version of PKLR gene
Designated 2022-02-24. Orphan designation status: Positive.
First published: 2022-05-13. Last updated: 2022-11-11.

Intended use: Treatment of pyruvate kinase deficiency

Source: https://www.ema.europa.eu/en/medicines/human/orphan-designations/eu-3-22-2580